EMA orphan designation EU/3/09/713
Active substance: veltuzumab
Designated 2010-01-29. Orphan designation status: Withdrawn.
First published: 2010-02-25. Last updated: 2020-03-06.

Intended use: Treatment of chronic lymphocytic leukaemia

Source: https://www.ema.europa.eu/en/medicines/human/orphan-designations/eu-3-09-713